EMA orphan designation EU/3/19/2167
Active substance: zanubrutinib
Designated 2019-05-29. Orphan designation status: Withdrawn.
First published: 2019-08-12. Last updated: 2021-12-15.

Intended use: Treatment of lymphoplasmacytic lymphoma

Source: https://www.ema.europa.eu/en/medicines/human/orphan-designations/eu-3-19-2167